EMA orphan designation EU/3/22/2657
Active substance: Salmonella enterica, subsp. enterica, serovar Typhimurium, strain YS1646, live
Designated 2022-07-18. Orphan designation status: Positive.
First published: 2023-01-23.

Intended use: Treatment of schwannoma

Source: https://www.ema.europa.eu/en/medicines/human/orphan-designations/eu-3-22-2657